EMA orphan designation EU/3/21/2550
Active substance: unesbulin
Designated 2021-12-10. Orphan designation status: Withdrawn.
First published: 2022-05-03. Last updated: 2025-04-09.

Intended use: Treatment of soft tissue sarcoma

Source: https://www.ema.europa.eu/en/medicines/human/orphan-designations/eu-3-21-2550